EMA orphan designation EU/3/18/2042
Active substance: adenovirus associated viral vector serotype 2/8 containing the human CNGA3 gene (entacingene turiparvovec)
Designated 2018-07-31. Orphan designation status: Positive.
First published: 2018-10-11. Last updated: 2024-10-22.

Intended use: Treatment of achromatopsia

Source: https://www.ema.europa.eu/en/medicines/human/orphan-designations/eu-3-18-2042